EMA orphan designation EU/3/04/186
Active substance: treosulfan
Designated 2004-02-22. Orphan designation status: Positive.
First published: 2004-11-22. Last updated: 2020-12-11.

Intended use: Conditioning treatment prior to haematopoietic-progenitor-cell transplantation

Source: https://www.ema.europa.eu/en/medicines/human/orphan-designations/eu-3-04-186